EMA orphan designation EU/3/17/1946: Voydeya
Active substance: (2S,4R)-1-(2-(3-acetyl-5-(2-methylpyrimidine-5-yl)-1H-indazol-1-yl)acetyl)-N-(6-bromopyridine-2-yl)-4-fluoropyrrolidine-2-carboxamide
Designated 2017-12-12. Orphan designation status: Positive. EMA product number: EMEA/H/C/005517.
First published: 2018-01-24. Last updated: 2020-06-08.

Intended use: Treatment of paroxysmal nocturnal haemoglobinuria

Source: https://www.ema.europa.eu/en/medicines/human/orphan-designations/eu-3-17-1946